EMA orphan designation EU/3/08/570
Active substance: recombinant human CXCL8 mutant
Designated 2008-09-22. Orphan designation status: Positive.
First published: 2009-04-02. Last updated: 2009-04-02.

Intended use: Prevention of delayed graft function after solid organ transplantation

Source: https://www.ema.europa.eu/en/medicines/human/orphan-designations/eu-3-08-570